EMA orphan designation EU/3/07/457
Active substance: Lusupultide
Designated 2007-07-10. Orphan designation status: Withdrawn.
First published: 2009-10-15. Last updated: 2009-10-15.

Intended use: Treatment of aspiration pneumonitis requiring intubation and mechanical ventilation

Source: https://www.ema.europa.eu/en/medicines/human/orphan-designations/eu-3-07-457